EMA orphan designation EU/3/24/2929
Active substance: afatinib
Designated 2024-06-28. Orphan designation status: Positive.
First published: 2024-08-22.

Intended use: Treatment of complex regional pain syndrome

Source: https://www.ema.europa.eu/en/medicines/human/orphan-designations/eu-3-24-2929